EMA orphan designation EU/3/17/1849
Active substance: adeno-associated viral vector serotype 8 containing the human alpha-galactosidase A gene
Designated 2017-03-20. Orphan designation status: Positive.
First published: 2017-05-05. Last updated: 2019-05-13.

Intended use: Treatment of Fabry disease

Source: https://www.ema.europa.eu/en/medicines/human/orphan-designations/eu-3-17-1849